EMA orphan designation EU/3/01/065
Active substance: Deoxyribose phosphorothioate (5'-tct-ccc-agc-gtg-cgc-cat-3')
Designated 2001-11-20. Orphan designation status: Withdrawn.
First published: 2005-12-12. Last updated: 2013-04-09.

Intended use: Treatment of multiple myeloma

Source: https://www.ema.europa.eu/en/medicines/human/orphan-designations/eu-3-01-065